EMA orphan designation EU/3/20/2252: Carvykti
Active substance: autologous human T cells genetically modified ex-vivo with a lentiviral vector encoding a chimeric antigen receptor for B-cell maturation antigen
Designated 2020-02-28. Orphan designation status: Positive. EMA product number: EMEA/H/C/005095.
First published: 2020-05-06. Last updated: 2022-06-13.

Intended use: Treatment of multiple myeloma

Source: https://www.ema.europa.eu/en/medicines/human/orphan-designations/eu-3-20-2252